EMA orphan designation EU/3/03/170
Active substance: human immunoglobulin
Designated 2003-10-24. Orphan designation status: Withdrawn.
First published: 2004-04-26. Last updated: 2013-10-08.

Intended use: Treatment of polymyositis

Source: https://www.ema.europa.eu/en/medicines/human/orphan-designations/eu-3-03-170